EMA orphan designation EU/3/05/323
Active substance: bacterial lipase (burlulipase)
Designated 2005-11-07. Orphan designation status: Positive.
First published: 2006-02-09. Last updated: 2021-06-21.

Intended use: Treatment of malabsorption due to exocrine pancreatic enzyme insufficiency

Source: https://www.ema.europa.eu/en/medicines/human/orphan-designations/eu-3-05-323